EMA orphan designation EU/3/15/1456
Active substance: recombinant human club cell 10 KDa protein
Designated 2015-03-19. Orphan designation status: Positive.
First published: 2015-05-11. Last updated: 2015-05-11.

Intended use: Prevention of bronchopulmonary dysplasia

Source: https://www.ema.europa.eu/en/medicines/human/orphan-designations/eu-3-15-1456